EMA orphan designation EU/3/12/1029
Active substance: Humanised monoclonal antibody against epidermal-growth-factor receptor
Designated 2012-08-09. Orphan designation status: Withdrawn.
First published: 2012-09-26. Last updated: 2013-09-23.

Intended use: Treatment of glioma

Source: https://www.ema.europa.eu/en/medicines/human/orphan-designations/eu-3-12-1029